EMA orphan designation EU/3/21/2503
Active substance: ganaxolone
Designated 2021-10-15. Orphan designation status: Positive.
First published: 2022-01-20. Last updated: 2025-06-05.

Intended use: Treatment of tuberous sclerosis

Source: https://www.ema.europa.eu/en/medicines/human/orphan-designations/eu-3-21-2503